EMA orphan designation EU/3/21/2462
Active substance: 1-(4-(6-chloropyridazin-3-yl)piperazin-1-yl)-2-(4-cyclopropyl-3-fluorophenyl)ethan-1-one
Designated 2021-07-19. Orphan designation status: Withdrawn.
First published: 2021-11-12. Last updated: 2025-06-10.

Intended use: Treatment of propionic acidaemia

Source: https://www.ema.europa.eu/en/medicines/human/orphan-designations/eu-3-21-2462